EMA orphan designation EU/3/21/2398
Active substance: 2'-O-methyl phosphorothioate RNA oligonucleotide, 5'-m5CUGm5CUGm5CUGm5CUGm5CUGm5CUGm5CUG-3'
Designated 2021-02-19. Orphan designation status: Positive.
First published: 2022-02-22.

Intended use: Treatment of spinocerebellar ataxia

Source: https://www.ema.europa.eu/en/medicines/human/orphan-designations/eu-3-21-2398